EMA orphan designation EU/3/21/2467
Active substance: sirolimus
Designated 2021-07-19. Orphan designation status: Positive.
First published: 2021-11-12. Last updated: 2021-12-21.

Intended use: Treatment of perivascular epithelioid cell tumours

Source: https://www.ema.europa.eu/en/medicines/human/orphan-designations/eu-3-21-2467